EMA orphan designation EU/3/09/710
Active substance: recombinant human elafin
Designated 2010-01-28. Orphan designation status: Positive.
First published: 2010-02-25. Last updated: 2023-06-20.

Intended use: Treatment of oesophagus carcinoma

Source: https://www.ema.europa.eu/en/medicines/human/orphan-designations/eu-3-09-710